EMA orphan designation EU/3/15/1554
Active substance: nimodipine
Designated 2015-10-09. Orphan designation status: Positive.
First published: 2015-11-16. Last updated: 2015-11-16.

Intended use: Treatment of aneurysmal subarachnoid haemorrhage

Source: https://www.ema.europa.eu/en/medicines/human/orphan-designations/eu-3-15-1554